EMA orphan designation EU/3/23/2871
Active substance: bersiporocin
Designated 2023-12-13. Orphan designation status: Positive.
First published: 2024-02-01.

Intended use: Treatment of idiopathic pulmonary fibrosis

Source: https://www.ema.europa.eu/en/medicines/human/orphan-designations/eu-3-23-2871